EMA orphan designation EU/3/17/1834
Active substance: Cyclo[L-alanyl-L-seryl-L-isoleucyl-L-prolyl-L-prolyl-L-glutaminyl-L-lysyl-L-tyrosyl-D-prolyl-L-prolyl-(2S)-2-aminodecanoyl-L-alpha-glutamyl-L-threonyl]acetate salt
Designated 2017-02-27. Orphan designation status: Positive.
First published: 2017-03-31. Last updated: 2018-06-14.

Intended use: Treatment of primary ciliary dyskinesia

Source: https://www.ema.europa.eu/en/medicines/human/orphan-designations/eu-3-17-1834